EMA orphan designation EU/3/24/2895
Active substance: tinengotinib
Designated 2024-02-19. Orphan designation status: Positive.
First published: 2024-04-11.

Intended use: Treatment of biliary tract cancer

Source: https://www.ema.europa.eu/en/medicines/human/orphan-designations/eu-3-24-2895